EMA orphan designation EU/3/11/924
Active substance: lenalidomide
Designated 2011-10-27. Orphan designation status: Withdrawn.
First published: 2011-11-23. Last updated: 2020-01-13.

Intended use: Treatment of mantle cell lymphoma

Source: https://www.ema.europa.eu/en/medicines/human/orphan-designations/eu-3-11-924